EMA orphan designation EU/3/15/1496
Active substance: trehalose
Designated 2015-05-21. Orphan designation status: Positive.
First published: 2015-06-30. Last updated: 2020-06-08.

Intended use: Treatment of oculopharyngeal muscular dystrophy

Source: https://www.ema.europa.eu/en/medicines/human/orphan-designations/eu-3-15-1496